EMA orphan designation EU/3/24/2964
Active substance: autologous CD34+ cells edited with a CRISPR/Cas9 system and transduced with an adeno-associated vector containing a codon-optimized version of WAS gene
Designated 2024-08-21. Orphan designation status: Positive.
First published: 2024-09-19.

Intended use: Treatment of Wiskott-Aldrich syndrome

Source: https://www.ema.europa.eu/en/medicines/human/orphan-designations/eu-3-24-2964